EMA orphan designation EU/3/25/3074
Active substance: (R)-3-(5-dimethylcarbamoyl-pent-1-enyl)-N-(2-hydroxy-1methylethyl)benzamide
Orphan designation status: Positive.
First published: 2025-07-17.

Intended use: Treatment of fragile X syndrome

Source: https://www.ema.europa.eu/en/medicines/human/orphan-designations/eu-3-25-3074